EMA orphan designation EU/3/04/220: Theraloc
Active substance: anti epidermal growth factor receptor antibody h-R3
Designated 2004-09-02. Orphan designation status: Positive. EMA product number: EMEA/H/C/000931.
First published: 2004-10-01. Last updated: 2018-10-12.

Intended use: Treatment of glioma

Source: https://www.ema.europa.eu/en/medicines/human/orphan-designations/eu-3-04-220